EMA orphan designation EU/3/14/1349
Active substance: recombinant human insulin receptor monoclonal antibody-fused-α-L-iduronidase
Designated 2014-10-15. Orphan designation status: Withdrawn.
First published: 2014-11-12. Last updated: 2022-02-18.

Intended use: Treatment of mucopolysaccharidosis type I

Source: https://www.ema.europa.eu/en/medicines/human/orphan-designations/eu-3-14-1349